EMA orphan designation EU/3/22/2678
Active substance: calmangafodipir
Designated 2022-08-10. Orphan designation status: Positive.
First published: 2022-12-15.

Intended use: Prevention of acute liver failure

Source: https://www.ema.europa.eu/en/medicines/human/orphan-designations/eu-3-22-2678